EMA orphan designation EU/3/12/953
Designated 2012-02-09. Orphan designation status: Withdrawn.
First published: 2012-03-01. Last updated: 2021-02-03.

Intended use: Treatment of West syndrome

Source: https://www.ema.europa.eu/en/medicines/human/orphan-designations/eu-3-12-953